EMA orphan designation EU/3/12/958
Active substance: N-[4-[[(2-amino-3,4-dihydro-4-oxo-6-pteridinyl)methyl]amino]benzoyl]-D-gamma-glutamyl-(2S)-2-amino-beta-alanyl-L-alpha-aspartyl-L-cysteine; folic acid
Designated 2012-02-09. Orphan designation status: Withdrawn.
First published: 2012-02-24. Last updated: 2012-11-12.

Intended use: Diagnosis of positive folate-receptor status in ovarian cancer

Source: https://www.ema.europa.eu/en/medicines/human/orphan-designations/eu-3-12-958